EMA orphan designation EU/3/08/592
Active substance: murine anti-CD22 antibody variable region fused to truncated Pseudomonas exotoxin 38 ( Moxetumomab pasudotox)
Designated 2008-12-05. Orphan designation status: Withdrawn.
First published: 2009-09-17. Last updated: 2021-08-19.

Intended use: Treatment of hairy cell leukaemia

Source: https://www.ema.europa.eu/en/medicines/human/orphan-designations/eu-3-08-592